EMA orphan designation EU/3/01/057
Active substance: Porcine lung surfactant
Designated 2001-09-19. Orphan designation status: Withdrawn.
First published: 2005-06-01. Last updated: 2005-06-01.

Intended use: Treatment of acute lung Injury

Source: https://www.ema.europa.eu/en/medicines/human/orphan-designations/eu-3-01-057